EMA orphan designation EU/3/16/1732: Qalsody
Active substance: synthetic ribonucleic acid oligonucleotide directed against superoxide dismutase 1 messenger ribonucleic acid (tofersen)
Designated 2016-08-29. Orphan designation status: Positive. EMA product number: EMEA/H/C/005493.
First published: 2016-10-03. Last updated: 2023-04-03.

Intended use: Treatment of amyotrophic lateral sclerosis

Source: https://www.ema.europa.eu/en/medicines/human/orphan-designations/eu-3-16-1732